EMA orphan designation EU/3/13/1155
Active substance: allogeneic motor neuron progenitor cells derived from human embryonic stem cells
Designated 2013-07-17. Orphan designation status: Positive.
First published: 2013-08-16. Last updated: 2013-08-16.

Intended use: Treatment of amyotrophic lateral sclerosis

Source: https://www.ema.europa.eu/en/medicines/human/orphan-designations/eu-3-13-1155